EMA orphan designation EU/3/16/1639
Active substance: (1E,6E)-1,7-bis(3,4-dimethoxyphenyl)-4-cyclobutylmethyl-1,6-heptadiene-3,5-dione
Designated 2016-04-28. Orphan designation status: Positive.
First published: 2016-05-31. Last updated: 2018-05-03.

Intended use: Treatment of X-linked spinal and bulbar muscular atrophy (Kennedy's disease)

Source: https://www.ema.europa.eu/en/medicines/human/orphan-designations/eu-3-16-1639